EMA orphan designation EU/3/21/2400
Active substance: anti-SIGLEC8 IgG1 humanised monoclonal antibody
Designated 2021-02-19. Orphan designation status: Withdrawn.
First published: 2022-02-22. Last updated: 2026-05-26.

Intended use: Treatment of eosinophilic gastroenteritis

Source: https://www.ema.europa.eu/en/medicines/human/orphan-designations/eu-3-21-2400